EMA orphan designation EU/3/12/987
Active substance: (E)-2,4,6-trimethoxystyryl-3-carboxymethylamino-4-methoxybenzyl-sulfone sodium salt (rigosertib sodium)
Designated 2012-04-26. Orphan designation status: Withdrawn.
First published: 2012-06-18. Last updated: 2021-10-27.

Intended use: Treatment of myelodysplastic syndromes

Source: https://www.ema.europa.eu/en/medicines/human/orphan-designations/eu-3-12-987